EMA orphan designation EU/3/23/2820
Active substance: Herpes simplex virus-1, derived from strain F, with deletions on genes gamma (1) 34.5 and UL39
Designated 2023-08-16. Orphan designation status: Positive.
First published: 2024-02-02.

Intended use: Treatment of glioma

Source: https://www.ema.europa.eu/en/medicines/human/orphan-designations/eu-3-23-2820